EMA orphan designation EU/3/23/2817
Active substance: alprostadil
Designated 2023-08-16. Orphan designation status: Positive.
First published: 2024-02-01.

Intended use: Treatment of in solid organ transplantation

Source: https://www.ema.europa.eu/en/medicines/human/orphan-designations/eu-3-23-2817